EMA orphan designation EU/3/25/3027
Active substance: tafasitamab
Designated 2025-02-26. Orphan designation status: Positive.
First published: 2025-03-11.

Intended use: Treatment of follicular lymphoma

Source: https://www.ema.europa.eu/en/medicines/human/orphan-designations/eu-3-25-3027